EMA orphan designation EU/3/07/498: Akantior
Active substance: Polihexanide
Designated 2007-11-14. Orphan designation status: Positive. EMA product number: EMEA/H/C/005858.
First published: 2008-07-02. Last updated: 2008-07-02.

Intended use: Treatment of acanthamoeba keratitis

Source: https://www.ema.europa.eu/en/medicines/human/orphan-designations/eu-3-07-498